EMA orphan designation EU/3/22/2737: Voranigo
Active substance: vorasidenib hemicitrate hemihydrate
Designated 2023-01-13. Orphan designation status: Positive. EMA product number: EMEA/H/C/006284.
First published: 2023-03-23.

Intended use: Treatment of glioma

Source: https://www.ema.europa.eu/en/medicines/human/orphan-designations/eu-3-22-2737